EMA orphan designation EU/3/07/502
Active substance: Picoplatin
Designated 2007-12-06. Orphan designation status: Withdrawn.
First published: 2008-07-02. Last updated: 2014-04-01.

Intended use: Treatment of small cell lung cancer

Source: https://www.ema.europa.eu/en/medicines/human/orphan-designations/eu-3-07-502